EMA orphan designation EU/3/16/1688
Active substance: setmelanotide
Designated 2016-06-27. Orphan designation status: Positive.
First published: 2016-07-26. Last updated: 2022-07-01.

Intended use: Treatment of Prader-Willi syndrome

Source: https://www.ema.europa.eu/en/medicines/human/orphan-designations/eu-3-16-1688